EMA orphan designation EU/3/25/3144
Active substance: fenfluramine hydrochloride
Designated 2025-10-20. Orphan designation status: Positive.
First published: 2026-04-14.

Intended use: Treatment of Rett syndrome

Source: https://www.ema.europa.eu/en/medicines/human/orphan-designations/eu-3-25-3144-0